EMA orphan designation EU/3/21/2556
Active substance: navtemadlin
Designated 2022-01-14. Orphan designation status: Positive.
First published: 2022-06-09.

Intended use: Treatment of Merkel cell carcinoma

Source: https://www.ema.europa.eu/en/medicines/human/orphan-designations/eu-3-21-2556